EMA orphan designation EU/3/25/3125
Active substance: temozolomide
Designated 2025-08-22. Orphan designation status: Positive.
First published: 2026-04-10.

Intended use: Treatment of glioma

Source: https://www.ema.europa.eu/en/medicines/human/orphan-designations/eu-3-25-3125